EMA orphan designation EU/3/25/3065
Active substance: bezuclastinib
Designated 2025-06-20. Orphan designation status: Positive.
First published: 2025-07-25.

Intended use: Treatment of mastocytosis

Source: https://www.ema.europa.eu/en/medicines/human/orphan-designations/eu-3-25-3065